EMA orphan designation EU/3/14/1422
Active substance: chimeric fusion protein of recombinant human alpha-N-acetylglucosaminidase and human insulin-like growth factor 2 (tralesinidase alfa)
Designated 2015-01-15. Orphan designation status: Positive.
First published: 2015-03-06. Last updated: 2022-01-14.

Intended use: Treatment of mucopolysaccharidosis type IIIB

Source: https://www.ema.europa.eu/en/medicines/human/orphan-designations/eu-3-14-1422